EMA orphan designation EU/3/21/2474
Active substance: autologous CD34+ hematopoietic stem and progenitor cells genetically modified with the lentiviral vector encoding for the human palmitoyl-protein thioesterase 1 gene
Designated 2021-07-19. Orphan designation status: Positive.
First published: 2021-11-16.

Intended use: Treatment of neuronal ceroid lipofuscinosis

Source: https://www.ema.europa.eu/en/medicines/human/orphan-designations/eu-3-21-2474